EMA orphan designation EU/3/05/263
Active substance: Dimethyl sulfoxide
Designated 2005-03-03. Orphan designation status: Positive.
First published: 2005-07-01. Last updated: 2022-02-04.

Intended use: Treatment of severe closed traumatic brain injury

Source: https://www.ema.europa.eu/en/medicines/human/orphan-designations/eu-3-05-263